EMA orphan designation EU/3/12/1098
Active substance: Encapsulated human retinal pigment epithelial cell line transfected with plasmid vector expressing human ciliary neurotrophic factor
Designated 2013-01-24. Orphan designation status: Positive.
First published: 2013-03-01. Last updated: 2022-04-12.

Intended use: Treatment of retinitis pigmentosa

Source: https://www.ema.europa.eu/en/medicines/human/orphan-designations/eu-3-12-1098